EMA orphan designation EU/3/18/2025
Active substance: modified mRNA encoding human methylmalonyl-coenzyme A mutase encapsulated into lipid nanoparticles
Designated 2018-05-25. Orphan designation status: Withdrawn.
First published: 2018-07-23. Last updated: 2023-06-26.

Intended use: Treatment of methylmalonic acidaemia

Source: https://www.ema.europa.eu/en/medicines/human/orphan-designations/eu-3-18-2025